EMA orphan designation EU/3/07/451
Active substance: cisplatin
Designated 2007-06-08. Orphan designation status: Positive.
First published: 2007-12-04. Last updated: 2007-12-04.

Intended use: Treatment of pancreatic cancer

Source: https://www.ema.europa.eu/en/medicines/human/orphan-designations/eu-3-07-451